EMA orphan designation EU/3/01/019
Active substance: Bosentan
Designated 2001-02-15. Orphan designation status: Expired.
First published: 2007-02-28. Last updated: 2013-02-27.

Intended use: Treatment of pulmonary arterial hypertension and chronic thromboembolic pulmonary hypertension

Source: https://www.ema.europa.eu/en/medicines/human/orphan-designations/eu-3-01-019